EMA orphan designation EU/3/12/972
Active substance: sialic acid (also known as aceneuramic acid)
Orphan designation status: Withdrawn.
First published: 2012-04-19. Last updated: 2018-06-13.

Intended use: Treatment of GNE myopathy

Source: https://www.ema.europa.eu/en/medicines/human/orphan-designations/eu-3-12-972